EMA orphan designation EU/3/18/2125
Active substance: benserazide hydrochloride
Designated 2019-01-11. Orphan designation status: Positive.
First published: 2019-03-27.

Intended use: The treatment of sickle cell disease

Source: https://www.ema.europa.eu/en/medicines/human/orphan-designations/eu-3-18-2125